EMA orphan designation EU/3/14/1334
Designated 2014-10-15. Orphan designation status: Positive.
First published: 2014-11-12. Last updated: 2014-11-12.

Intended use: Treatment of fragile X syndrome

Source: https://www.ema.europa.eu/en/medicines/human/orphan-designations/eu-3-14-1334